EMA orphan designation EU/3/18/2007
Active substance: adeno-associated viral vector serotype 8 containing the human acid alpha-glucosidase gene
Orphan designation status: Withdrawn.
First published: 2017-05-17. Last updated: 2026-05-26.

Intended use: Treatment of glycogen storage disease type II (Pompe's disease)

Source: https://www.ema.europa.eu/en/medicines/human/orphan-designations/eu-3-18-2007